EMA orphan designation EU/3/17/1863: Abecma
Active substance: autologous T lymphocyte-enriched population of cells transduced with a lentiviral vector encoding a chimeric antigen receptor targeting human B cell maturation antigen with 4-1BB and CD3-zeta intracellular signalling domains (idecabtagene vicleucel)
Designated 2017-04-20. Orphan designation status: Positive. EMA product number: EMEA/H/C/004662.
First published: 2017-05-15. Last updated: 2021-12-03.

Intended use: Treatment of multiple myeloma

Source: https://www.ema.europa.eu/en/medicines/human/orphan-designations/eu-3-17-1863